EMA orphan designation EU/3/20/2312
Active substance: allogeneic hepatoblastoma cells encapsulated in alginate, ex vivo expanded
Designated 2020-08-21. Orphan designation status: Positive.
First published: 2020-11-25. Last updated: 2021-06-24.

Intended use: Treatment of acute liver failure

Source: https://www.ema.europa.eu/en/medicines/human/orphan-designations/eu-3-20-2312